EMA orphan designation EU/3/21/2525
Active substance: Ibrexafungerp
Designated 2021-11-12. Orphan designation status: Positive.
First published: 2022-06-09. Last updated: 2024-10-02.

Intended use: Treatment of invasive candidiasis

Source: https://www.ema.europa.eu/en/medicines/human/orphan-designations/eu-3-21-2525